EMA orphan designation EU/3/13/1111
Active substance: Gevokizumab
Designated 2013-03-12. Orphan designation status: Withdrawn.
First published: 2013-04-05. Last updated: 2018-07-18.

Intended use: Treatment of chronic non-infectious uveitis

Source: https://www.ema.europa.eu/en/medicines/human/orphan-designations/eu-3-13-1111